EMA orphan designation EU/3/10/830
Active substance: veliparib
Designated 2010-12-17. Orphan designation status: Withdrawn.
First published: 2011-01-20. Last updated: 2024-04-09.

Intended use: Treatment of ovarian cancer

Source: https://www.ema.europa.eu/en/medicines/human/orphan-designations/eu-3-10-830